EMA orphan designation EU/3/19/2166
Active substance: sodium benzoate
Designated 2019-05-29. Orphan designation status: Positive.
First published: 2019-08-12.

Intended use: Treatment of carbamoyl-phosphate synthase-1 deficiency

Source: https://www.ema.europa.eu/en/medicines/human/orphan-designations/eu-3-19-2166